EMA orphan designation EU/3/04/249
Active substance: recombinant histidine-tagged idiotype immunoglobulin Fab fragment of clonal B-cell receptors
Designated 2004-12-23. Orphan designation status: Positive.
First published: 2006-01-06. Last updated: 2011-05-31.

Intended use: Treatment of follicular lymphoma

Source: https://www.ema.europa.eu/en/medicines/human/orphan-designations/eu-3-04-249